EMA orphan designation EU/3/18/2037: Skyclarys
Active substance: omaveloxolone
Designated 2018-06-27. Orphan designation status: Positive. EMA product number: EMEA/H/C/006084.
First published: 2018-08-22. Last updated: 2024-10-22.

Intended use: Treatment of Friedreich’s ataxia

Source: https://www.ema.europa.eu/en/medicines/human/orphan-designations/eu-3-18-2037